EMA orphan designation EU/3/20/2266
Active substance: DNA plasmid encoding IL-12 p35 and p40 genes
Designated 2020-04-22. Orphan designation status: Positive.
First published: 2020-08-11.

Intended use: Treatment of ovarian cancer

Source: https://www.ema.europa.eu/en/medicines/human/orphan-designations/eu-3-20-2266